EMA orphan designation EU/3/20/2276
Active substance: methotrexate
Designated 2020-06-04. Orphan designation status: Positive.
First published: 2020-08-19.

Intended use: Treatment of retinal detachment

Source: https://www.ema.europa.eu/en/medicines/human/orphan-designations/eu-3-20-2276